EMA orphan designation EU/3/21/2504
Active substance: mocravimod
Designated 2021-10-15. Orphan designation status: Positive.
First published: 2022-01-20. Last updated: 2022-04-13.

Intended use: Treatment in haematopoietic stem cell transplantation

Source: https://www.ema.europa.eu/en/medicines/human/orphan-designations/eu-3-21-2504